EMA orphan designation EU/3/16/1745
Active substance: autologous T cells transduced with lentiviral vector containing a chimeric antigen receptor directed against CD19 (tisagenlecleucel)
Designated 2016-10-14. Orphan designation status: Positive.
First published: 2016-11-14. Last updated: 2018-06-13.

Intended use: Treatment of diffuse large B-cell lymphoma

Source: https://www.ema.europa.eu/en/medicines/human/orphan-designations/eu-3-16-1745